EMA orphan designation EU/3/25/3092
Active substance: curcumin
Designated 2025-07-18. Orphan designation status: Positive.
First published: 2025-09-04.

Intended use: Treatment of Charcot-Marie-Tooth disease

Source: https://www.ema.europa.eu/en/medicines/human/orphan-designations/eu-3-25-3092